EMA orphan designation EU/3/06/386
Active substance: 4-[123I] Iodo-L-phenylalanine
Designated 2006-07-25. Orphan designation status: Positive.
First published: 2009-04-24. Last updated: 2009-04-24.

Intended use: Diagnosis of glioma

Source: https://www.ema.europa.eu/en/medicines/human/orphan-designations/eu-3-06-386